EMA orphan designation EU/3/04/224
Active substance: Homoharringtonine
Designated 2004-09-02. Orphan designation status: Withdrawn.
First published: 2009-09-21. Last updated: 2016-08-10.

Intended use: Treatment of chronic myeloid leukaemia

Source: https://www.ema.europa.eu/en/medicines/human/orphan-designations/eu-3-04-224